EMA orphan designation EU/3/14/1285
Active substance: afamelanotide
Designated 2014-07-04. Orphan designation status: Positive.
First published: 2014-07-15. Last updated: 2016-04-11.

Intended use: Treatment of familial benign chronic pemphigus (Hailey-Hailey disease)

Source: https://www.ema.europa.eu/en/medicines/human/orphan-designations/eu-3-14-1285